EMA orphan designation EU/3/25/3102
Active substance: mecasermin rinfabate
Designated 2025-08-22. Orphan designation status: Positive.
First published: 2026-04-10.

Intended use: Prevention of bronchopulmonary dysplasia

Source: https://www.ema.europa.eu/en/medicines/human/orphan-designations/eu-3-25-3102